EMA orphan designation EU/3/10/766
Active substance: Pyr-His-Trp-Ser-Tyr-D-Lys(doxorubicinylglutarate)-Leu-Arg-Pro-Gly-NH2, acetate salt
Designated 2010-08-04. Orphan designation status: Withdrawn.
First published: 2010-08-11. Last updated: 2017-08-16.

Intended use: Treatment of ovarian cancer

Source: https://www.ema.europa.eu/en/medicines/human/orphan-designations/eu-3-10-766